EMA orphan designation EU/3/22/2600
Active substance: cannabidiol
Designated 2022-04-13. Orphan designation status: Positive.
First published: 2022-07-19. Last updated: 2023-06-16.

Intended use: Treatment of epilepsy with myoclonic-atonic seizures

Source: https://www.ema.europa.eu/en/medicines/human/orphan-designations/eu-3-22-2600